EMA orphan designation EU/3/11/897
Active substance: N-{[(5S)-3-(3-fluoro-4-thiomorpholin-4-ylphenyl)-2-oxo-1,3-oxazolidin-5-yl]methyl}acetamide
Designated 2011-08-30. Orphan designation status: Positive.
First published: 2011-09-21. Last updated: 2015-03-12.

Intended use: Treatment of tuberculosis

Source: https://www.ema.europa.eu/en/medicines/human/orphan-designations/eu-3-11-897